EMA orphan designation EU/3/14/1403
Active substance: Plerixafor
Designated 2014-12-16. Orphan designation status: Positive.
First published: 2015-02-27. Last updated: 2015-02-27.

Intended use: Treatment of WHIM syndrome

Source: https://www.ema.europa.eu/en/medicines/human/orphan-designations/eu-3-14-1403